EMA orphan designation EU/3/18/2026: Amvuttra
Active substance: synthetic double-stranded siRNA oligonucleotide targeted against transthyretin mRNA, with six phosphorothioate linkages in the backbone, and nine 2'-fluoro and thirty-five 2'-O-methyl nucleoside residues in the sequence, which is covalently linked via a phosphodiester group to a ligand containing three N-acetylgalactosamine residues (Vutrisiran)
Designated 2018-05-25. Orphan designation status: Positive. EMA product number: EMEA/H/C/005852.
First published: 2018-07-23. Last updated: 2025-07-17.

Intended use: Treatment of transthyretin-mediated amyloidosis (ATTR amyloidosis)

Source: https://www.ema.europa.eu/en/medicines/human/orphan-designations/eu-3-18-2026